EMA orphan designation EU/3/11/853
Active substance: ombrabulin
Designated 2011-04-15. Orphan designation status: Withdrawn.
First published: 2011-04-26. Last updated: 2014-06-19.

Intended use: Treatment of soft tissue sarcoma

Source: https://www.ema.europa.eu/en/medicines/human/orphan-designations/eu-3-11-853